EMA orphan designation EU/3/17/1936: Qinlock
Active substance: 1-[4-bromo-5-[1-ethyl-7-(methylamino)-2-oxo-1,2-dihydro-1,6-naphthyridin-3-yl]-2-fluorophenyl]-3-phenylurea
Designated 2017-11-08. Orphan designation status: Positive. EMA product number: EMEA/H/C/005614.
First published: 2018-01-15. Last updated: 2021-11-23.

Intended use: Treatment of gastrointestinal stromal tumours

Source: https://www.ema.europa.eu/en/medicines/human/orphan-designations/eu-3-17-1936